EMA orphan designation EU/3/15/1512
Active substance: anti-H5N1 equine immunoglobulin F(ab')2 fragments
Designated 2015-07-28. Orphan designation status: Positive.
First published: 2015-08-18. Last updated: 2015-08-18.

Intended use: Treatment of avian influenza

Source: https://www.ema.europa.eu/en/medicines/human/orphan-designations/eu-3-15-1512